EMA orphan designation EU/3/12/970
Active substance: 6-ethynyl-1-(pentan-3-yl)-1H-imidazo[4,5-b]pyrazin-2(3H)-one (tirasemtiv)
Designated 2012-03-05. Orphan designation status: Positive.
First published: 2012-04-19. Last updated: 2024-10-11.

Intended use: Treatment of amyotrophic lateral sclerosis

Source: https://www.ema.europa.eu/en/medicines/human/orphan-designations/eu-3-12-970